EMA orphan designation EU/3/24/2927
Active substance: adeno-associated virus serotype rh.74 vector containing the human PKP2 gene isoform
Designated 2024-05-24. Orphan designation status: Positive.
First published: 2024-08-22.

Intended use: Treatment of arrhythmogenic cardiomyopathy caused by pathogenic mutations in the PKP2 gene

Source: https://www.ema.europa.eu/en/medicines/human/orphan-designations/eu-3-24-2927